EMA orphan designation EU/3/24/2995
Active substance: Curcumin E100, Resveratrol
Designated 2024-11-11. Orphan designation status: Positive.
First published: 2024-11-21.

Intended use: Treatment of Dercum disease

Source: https://www.ema.europa.eu/en/medicines/human/orphan-designations/eu-3-24-2995